EMA orphan designation EU/3/07/488
Active substance: everolimus
Designated 2007-11-14. Orphan designation status: Withdrawn.
First published: 2008-07-02. Last updated: 2015-02-09.

Intended use: Treatment of gastro-entero-pancreatic neuroendocrine tumours

Source: https://www.ema.europa.eu/en/medicines/human/orphan-designations/eu-3-07-488